EMA orphan designation EU/3/03/167
Active substance: H-Tyrosine-Glycine-Phenylalanine-Glycine-Glycine-OH
Designated 2003-10-20. Orphan designation status: Withdrawn.
First published: 2009-10-15. Last updated: 2009-10-15.

Intended use: Treatment of chronic idiopathic myelofibrosis

Source: https://www.ema.europa.eu/en/medicines/human/orphan-designations/eu-3-03-167